EMA orphan designation EU/3/15/1495
Active substance: triheptanoin
Designated 2015-05-21. Orphan designation status: Withdrawn.
First published: 2015-06-30. Last updated: 2020-01-16.

Intended use: Treatment of glucose transporter type-1 deficiency syndrome

Source: https://www.ema.europa.eu/en/medicines/human/orphan-designations/eu-3-15-1495